EMA orphan designation EU/3/13/1187
Active substance: Idelalisib
Designated 2013-08-05. Orphan designation status: Withdrawn.
First published: 2013-09-16. Last updated: 2013-11-18.

Intended use: Treatment of splenic marginal-zone lymphoma

Source: https://www.ema.europa.eu/en/medicines/human/orphan-designations/eu-3-13-1187